EMA orphan designation EU/3/03/159
Active substance: recombinant human insulin-like growth factor-I; recombinant human insulin-like growth factor binding protein-3
Designated 2003-07-09. Orphan designation status: Withdrawn.
First published: 2007-05-30. Last updated: 2011-06-21.

Intended use: Treatment of primary growth hormone insensitivity syndrome (laron syndrome)

Source: https://www.ema.europa.eu/en/medicines/human/orphan-designations/eu-3-03-159